EMA orphan designation EU/3/16/1703: Imcivree
Active substance: setmelanotide
Designated 2016-07-14. Orphan designation status: Positive. EMA product number: EMEA/H/C/005089.
First published: 2016-09-06. Last updated: 2022-07-01.

Intended use: Treatment of pro-opiomelanocortin deficiency

Source: https://www.ema.europa.eu/en/medicines/human/orphan-designations/eu-3-16-1703